EMA orphan designation EU/3/21/2470
Active substance: pridopidine hydrochloride
Designated 2021-07-19. Orphan designation status: Positive.
First published: 2021-11-12. Last updated: 2026-05-11.

Intended use: Treatment of amyotrophic lateral sclerosis

Source: https://www.ema.europa.eu/en/medicines/human/orphan-designations/eu-3-21-2470